EMA orphan designation EU/3/19/2140
Active substance: Humanised IGg1 monoclonal antibody targeting human transferrin receptor conjugated to human iduronate-2-sulfatase
Designated 2019-02-26. Orphan designation status: Positive.
First published: 2019-04-09. Last updated: 2025-06-10.

Intended use: Treatment of mucopolysaccharidosis type II (Hunter's syndrome)

Source: https://www.ema.europa.eu/en/medicines/human/orphan-designations/eu-3-19-2140